EMA orphan designation EU/3/10/815
Active substance: sildenafil citrate
Designated 2010-11-26. Orphan designation status: Positive.
First published: 2010-12-17. Last updated: 2018-10-23.

Intended use: treatment of postcardiotomy right ventricular failure

Source: https://www.ema.europa.eu/en/medicines/human/orphan-designations/eu-3-10-815